EMA orphan designation EU/3/12/992
Active substance: exon 53 specific phosphorothioate oligonucleotide
Designated 2012-04-26. Orphan designation status: Positive.
First published: 2012-06-18. Last updated: 2016-12-05.

Intended use: Treatment of Duchenne muscular dystrophy

Source: https://www.ema.europa.eu/en/medicines/human/orphan-designations/eu-3-12-992